EMA orphan designation EU/3/13/1232
Active substance: allantoin
Designated 2014-01-16. Orphan designation status: Withdrawn.
First published: 2014-03-24. Last updated: 2020-12-10.

Intended use: Treatment of epidermolysis bullosa

Source: https://www.ema.europa.eu/en/medicines/human/orphan-designations/eu-3-13-1232